EMA orphan designation EU/3/20/2257
Active substance: sintilimab
Designated 2020-02-28. Orphan designation status: Positive.
First published: 2020-05-06.

Intended use: Treatment of peripheral T-cell lymphoma

Source: https://www.ema.europa.eu/en/medicines/human/orphan-designations/eu-3-20-2257